EMA orphan designation EU/3/14/1430
Active substance: adeno-associated viral vector serotype 8 containing the human factor-VII gene
Designated 2015-01-15. Orphan designation status: Positive.
First published: 2015-03-06. Last updated: 2020-03-05.

Intended use: Treatment of congenital factor VII deficiency

Source: https://www.ema.europa.eu/en/medicines/human/orphan-designations/eu-3-14-1430